EMA orphan designation EU/3/24/3018
Active substance: alvelestat
Designated 2025-01-16. Orphan designation status: Positive.
First published: 2025-02-20.

Intended use: Treatment of congenital alpha-1 antitrypsin deficiency

Source: https://www.ema.europa.eu/en/medicines/human/orphan-designations/eu-3-24-3018